EMA orphan designation EU/3/01/084
Active substance: azacitidine
Designated 2002-02-06. Orphan designation status: Expired.
First published: 2009-09-17. Last updated: 2019-02-20.

Intended use: Treatment of myelodysplastic syndromes

Source: https://www.ema.europa.eu/en/medicines/human/orphan-designations/eu-3-01-084